EMA orphan designation EU/3/21/2415
Active substance: Lefitolimod
Designated 2021-03-26. Orphan designation status: Positive.
First published: 2022-01-19. Last updated: 2022-05-19.

Intended use: Treatment of small cell lung cancer

Source: https://www.ema.europa.eu/en/medicines/human/orphan-designations/eu-3-21-2415